EMA orphan designation EU/3/20/2302
Active substance: triheptanoin
Designated 2020-07-27. Orphan designation status: Positive.
First published: 2020-11-19.

Intended use: Treatment of carnitine-acylcarnitine translocase deficiency

Source: https://www.ema.europa.eu/en/medicines/human/orphan-designations/eu-3-20-2302